EMA orphan designation EU/3/20/2295
Active substance: Lys40(NODAGA-68Ga)NH2-exendin-4
Designated 2020-06-26. Orphan designation status: Positive.
First published: 2020-09-24.

Intended use: Diagnosis of insulinoma

Source: https://www.ema.europa.eu/en/medicines/human/orphan-designations/eu-3-20-2295